EMA orphan designation EU/3/13/1099
Active substance: recombinant adeno-associated viral vector containing the human CNGB3 gene
Designated 2013-02-08. Orphan designation status: Positive.
First published: 2013-03-04. Last updated: 2023-06-16.

Intended use: Treatment of achromatopsia caused by mutations in the CNGB3 gene

Source: https://www.ema.europa.eu/en/medicines/human/orphan-designations/eu-3-13-1099